EMA orphan designation EU/3/15/1434
Active substance: chimeric group B adenovirus (11p/3) with deletions in the E3 and E4 regions (enadenotucirev)
Designated 2015-02-12. Orphan designation status: Withdrawn.
First published: 2015-04-08. Last updated: 2021-06-25.

Intended use: Treatment of ovarian cancer

Source: https://www.ema.europa.eu/en/medicines/human/orphan-designations/eu-3-15-1434